EMA orphan designation EU/3/21/2429: Ifinwil
Active substance: eflornithine
Designated 2021-05-20. Orphan designation status: Positive. EMA product number: EMEA/H/C/006067.
First published: 2022-03-17.

Intended use: Treatment of neuroblastoma

Source: https://www.ema.europa.eu/en/medicines/human/orphan-designations/eu-3-21-2429